EMA orphan designation EU/3/15/1556
Active substance: recombinant adeno-associated viral vector expressing the human CNGA3 gene
Designated 2015-10-09. Orphan designation status: Positive.
First published: 2015-11-16. Last updated: 2023-06-27.

Intended use: Treatment of achromatopsia caused by mutations in the CNGA3 gene

Source: https://www.ema.europa.eu/en/medicines/human/orphan-designations/eu-3-15-1556